EMA orphan designation EU/3/24/2969
Active substance: Humanised IgG1 monoclonal antibody against misfolded immunoglobulin G, fused with pan-amyloid-reactive peptide p5R
Orphan designation status: Positive.
First published: 2024-09-19.

Intended use: Treatment of AL amyloidosis

Source: https://www.ema.europa.eu/en/medicines/human/orphan-designations/eu-3-24-2969